EMA orphan designation EU/3/22/2702
Active substance: human IgG4k monoclonal antibody against CD89
Designated 2022-10-11. Orphan designation status: Positive.
First published: 2023-01-24.

Intended use: Treatment of linear IgA bullous dermatosis

Source: https://www.ema.europa.eu/en/medicines/human/orphan-designations/eu-3-22-2702